EMA orphan designation EU/3/17/1963
Active substance: hydroxychloroquine sulfate
Orphan designation status: Positive.
First published: 2018-01-17. Last updated: 2018-01-17.

Intended use: Treatment of LIPIN1 disease

Source: https://www.ema.europa.eu/en/medicines/human/orphan-designations/eu-3-17-1963